EMA orphan designation EU/3/25/3109
Active substance: adeno-associated virus serotype 9 vector containing the human NDP gene
Designated 2025-08-22. Orphan designation status: Positive.
First published: 2026-04-10.

Intended use: Treatment of NDP gene-related disorders

Source: https://www.ema.europa.eu/en/medicines/human/orphan-designations/eu-3-25-3109